EMA orphan designation EU/3/23/2763
Active substance: Fosmanogepix
Designated 2023-03-20. Orphan designation status: Positive.
First published: 2024-02-01. Last updated: 2024-10-02.

Intended use: Treatment of scedosporiosis

Source: https://www.ema.europa.eu/en/medicines/human/orphan-designations/eu-3-23-2763